EMA orphan designation EU/3/12/991
Active substance: exon 45 specific phosphorothioate oligonucleotide
Designated 2012-04-26. Orphan designation status: Positive.
First published: 2012-06-18. Last updated: 2016-12-05.

Intended use: Treatment of Duchenne muscular dystrophy

Source: https://www.ema.europa.eu/en/medicines/human/orphan-designations/eu-3-12-991